EMA orphan designation EU/3/26/3204
Active substance: N-[(1S)-1-(4-Tert-butylphenyl) ethyl]-2-(6,7-difluoro-1H-benzimidazol-1-yl)acetamide
Designated 2026-02-23. Orphan designation status: Positive.
First published: 2026-04-10.

Intended use: Treatment of erythromelalgia

Source: https://www.ema.europa.eu/en/medicines/human/orphan-designations/eu-3-26-3204